EMA orphan designation EU/3/14/1308
Active substance: sodium ascorbate; menadione sodium bisulfite
Designated 2014-08-22. Orphan designation status: Positive.
First published: 2014-09-29. Last updated: 2016-08-16.

Intended use: Treatment of autosomal dominant polycystic liver disease

Source: https://www.ema.europa.eu/en/medicines/human/orphan-designations/eu-3-14-1308